EMA orphan designation EU/3/20/2311
Active substance: sodium (4-{(E)-3-(4-fluorophenyl)-3-[4-(3-morpholin-4-yl-prop1ynyl)phenyl]allyloxy}-2-methylphenoxy)acetate
Designated 2020-08-21. Orphan designation status: Withdrawn.
First published: 2020-11-25. Last updated: 2024-04-11.

Intended use: Treatment of mitochondrial encephalomyopathy, lactic acidosis and stroke-like episodes

Source: https://www.ema.europa.eu/en/medicines/human/orphan-designations/eu-3-20-2311